EMA orphan designation EU/3/10/790
Active substance: ambrisentan
Designated 2010-10-01. Orphan designation status: Withdrawn.
First published: 2010-10-14. Last updated: 2011-09-05.

Intended use: Treatment idiopathic pulmonary fibrosis

Source: https://www.ema.europa.eu/en/medicines/human/orphan-designations/eu-3-10-790